EMA orphan designation EU/3/10/826
Active substance: para-aminosalicylic acid
Designated 2010-12-17. Orphan designation status: Positive.
First published: 2011-01-20. Last updated: 2018-06-08.

Intended use: Treatment of tuberculosis

Source: https://www.ema.europa.eu/en/medicines/human/orphan-designations/eu-3-10-826